EMA orphan designation EU/3/21/2435: Sephience
Active substance: (S)-2-amino-6-(2-hydroxypropanoyl)-7,8-dihydropteridin-4(3H)-one
Designated 2021-05-20. Orphan designation status: Positive. EMA product number: EMEA/H/C/006331.
First published: 2022-03-18. Last updated: 2025-04-25.

Intended use: Treatment of hyperphenylalaninemia

Source: https://www.ema.europa.eu/en/medicines/human/orphan-designations/eu-3-21-2435